EMA orphan designation EU/3/14/1305
Active substance: Humanised recombinant monoclonal antibody against epidermal growth factor receptor conjugated to maleimidocaproyl monomethylauristatin F(depatuxizumab mafodotin
Designated 2014-07-29. Orphan designation status: Withdrawn.
First published: 2014-09-01. Last updated: 2019-10-15.

Intended use: Treatment of glioma

Source: https://www.ema.europa.eu/en/medicines/human/orphan-designations/eu-3-14-1305